EMA orphan designation EU/3/15/1471
Active substance: trientine tetrahydrochloride
Designated 2015-03-19. Orphan designation status: Withdrawn.
First published: 2015-05-19. Last updated: 2017-10-03.

Intended use: Treatment of Wilson's disease

Source: https://www.ema.europa.eu/en/medicines/human/orphan-designations/eu-3-15-1471